EMA orphan designation EU/3/22/2662
Active substance: vilobelimab
Designated 2022-07-18. Orphan designation status: Positive.
First published: 2023-01-23.

Intended use: Treatment of pyoderma gangrenosum

Source: https://www.ema.europa.eu/en/medicines/human/orphan-designations/eu-3-22-2662